EMA orphan designation EU/3/07/459: Wakix
Active substance: 1-{3-[3-(4-Chlorophenyl)propoxy]propyl}piperidine, hydrochloride
Designated 2007-07-10. Orphan designation status: Positive. EMA product number: EMEA/H/C/002616.
First published: 2007-12-04. Last updated: 2016-04-20.

Intended use: Treatment of narcolepsy

Source: https://www.ema.europa.eu/en/medicines/human/orphan-designations/eu-3-07-459